EMA orphan designation EU/3/15/1539
Active substance: adeno-associated viral vector serotype 8 containing the human MTM1 gene (resamirigene bilparvovec)
Designated 2015-08-10. Orphan designation status: Positive.
First published: 2015-10-02. Last updated: 2020-09-18.

Intended use: Treatment of X-linked myotubular myopathy

Source: https://www.ema.europa.eu/en/medicines/human/orphan-designations/eu-3-15-1539